EMA orphan designation EU/3/15/1508
Active substance: triheptanoin
Designated 2015-06-19. Orphan designation status: Positive.
First published: 2015-07-27. Last updated: 2020-11-11.

Intended use: Treatment of very long-chain acyl-CoA dehydrogenase deficiency

Source: https://www.ema.europa.eu/en/medicines/human/orphan-designations/eu-3-15-1508